EMA orphan designation EU/3/04/233
Active substance: adeno-associated viral vector containing the human gamma-sarcoglycan gene
Designated 2004-10-21. Orphan designation status: Withdrawn.
First published: 2005-10-11. Last updated: 2014-06-18.

Intended use: Treatment of gamma sarcoglycanopathy

Source: https://www.ema.europa.eu/en/medicines/human/orphan-designations/eu-3-04-233